EMA orphan designation EU/3/17/1942
Active substance: N-(2-aminophenyl)-4-(1-[(1,3-dimethyl-1H-pyrazol-4-yl)methyl]piperidin)benzamide
Designated 2017-10-12. Orphan designation status: Positive.
First published: 2018-01-15. Last updated: 2018-01-15.

Intended use: Treatment of peripheral T-cell lymphoma

Source: https://www.ema.europa.eu/en/medicines/human/orphan-designations/eu-3-17-1942